EMA orphan designation EU/3/16/1669
Active substance: temsirolimus
Designated 2016-05-30. Orphan designation status: Positive.
First published: 2016-06-24. Last updated: 2022-11-11.

Intended use: Treatment of adrenoleukodystrophy

Source: https://www.ema.europa.eu/en/medicines/human/orphan-designations/eu-3-16-1669